EMA orphan designation EU/3/18/2112
Active substance: Bifunctional fusion protein composed of two extracellular domains of transforming growth factor beta receptor II fused with a human immunoglobulin G1 monoclonal antibody against programmed death ligand 1
Designated 2018-12-14. Orphan designation status: Withdrawn.
First published: 2019-03-11. Last updated: 2022-02-07.

Intended use: Treatment of biliary tract cancer

Source: https://www.ema.europa.eu/en/medicines/human/orphan-designations/eu-3-18-2112